EMA orphan designation EU/3/12/1016
Active substance: 16-base single-stranded peptide nucleic acid oligonucleotide linked to 7-amino acid peptide
Designated 2012-07-04. Orphan designation status: Positive.
First published: 2012-07-24. Last updated: 2014-04-01.

Intended use: Treatment of neuroblastoma

Source: https://www.ema.europa.eu/en/medicines/human/orphan-designations/eu-3-12-1016